EMA orphan designation EU/3/21/2483
Active substance: human keratinocytes
Designated 2021-08-20. Orphan designation status: Positive.
First published: 2022-02-25.

Intended use: Treatment of partial deep dermal and full thickness burns

Source: https://www.ema.europa.eu/en/medicines/human/orphan-designations/eu-3-21-2483